EMA orphan designation EU/3/18/1983
Active substance: pyridoxal 5'-phosphate
Designated 2018-02-22. Orphan designation status: Positive.
First published: 2018-04-11. Last updated: 2018-04-11.

Intended use: Treatment of pyridoxamine 5'-phosphate oxidase deficiency

Source: https://www.ema.europa.eu/en/medicines/human/orphan-designations/eu-3-18-1983